EMA orphan designation EU/3/05/274
Active substance: melatonin
Designated 2005-04-11. Orphan designation status: Withdrawn.
First published: 2005-10-27. Last updated: 2014-06-19.

Intended use: Treatment of non-24-hour sleep-wake disorders in blind people with no light perception

Source: https://www.ema.europa.eu/en/medicines/human/orphan-designations/eu-3-05-274